EMA orphan designation EU/3/01/079
Active substance: sinapultide; Dipalmitoylphosphatidylcholine; palmitoyloleoyl phosphatidylglycerol; palmitic acid
Designated 2002-02-04. Orphan designation status: Positive.
First published: 2007-05-30. Last updated: 2019-05-22.

Intended use: Treatment of acute lung Injury

Source: https://www.ema.europa.eu/en/medicines/human/orphan-designations/eu-3-01-079